EMA orphan designation EU/3/15/1588
Active substance: (R)-1-[1-(4-acetoxy-3,3-dimethyl-2-oxo-butyl)-2-oxo-5-(pyridin-2-yl)-2,3-dihydro-1H-benzo[e][1,4]diazepin-3-yl]-3-(3-methylamino-phenyl)-urea (ceclazepide)
Designated 2015-12-14. Orphan designation status: Withdrawn.
First published: 2016-02-02. Last updated: 2023-04-04.

Intended use: Treatment of gastro-entero-pancreatic neuroendocrine tumours

Source: https://www.ema.europa.eu/en/medicines/human/orphan-designations/eu-3-15-1588